EMA orphan designation EU/3/24/3008
Active substance: diazoxide choline
Designated 2024-12-13. Orphan designation status: Positive.
First published: 2025-01-15.

Intended use: Treatment of glycogen storage disease type I

Source: https://www.ema.europa.eu/en/medicines/human/orphan-designations/eu-3-24-3008-0